EMA orphan designation EU/3/17/1878
Active substance: ursodeoxycholic acid
Designated 2017-05-22. Orphan designation status: Positive.
First published: 2017-06-29. Last updated: 2019-04-25.

Intended use: Treatment of Niemann-Pick disease

Source: https://www.ema.europa.eu/en/medicines/human/orphan-designations/eu-3-17-1878